EMA orphan designation EU/3/05/330
Active substance: tilarginine acetate
Designated 2005-11-03. Orphan designation status: Withdrawn.
First published: 2009-10-15. Last updated: 2009-10-15.

Intended use: Treatment of cardiogenic shock

Source: https://www.ema.europa.eu/en/medicines/human/orphan-designations/eu-3-05-330